EMA orphan designation EU/3/07/469
Active substance: ciprofloxacin
Designated 2007-08-03. Orphan designation status: Withdrawn.
First published: 2009-06-15. Last updated: 2019-02-21.

Intended use: Treatment of cystic fibrosis

Source: https://www.ema.europa.eu/en/medicines/human/orphan-designations/eu-3-07-469